EMA orphan designation EU/3/17/1866
Active substance: human normal immunoglobulin
Designated 2017-04-20. Orphan designation status: Positive.
First published: 2017-05-15. Last updated: 2017-05-15.

Intended use: Treatment in solid organ transplantation

Source: https://www.ema.europa.eu/en/medicines/human/orphan-designations/eu-3-17-1866